EMA orphan designation EU/3/08/551
Active substance: pegylated recombinant factor VIIa
Designated 2008-06-04. Orphan designation status: Withdrawn.
First published: 2008-08-18. Last updated: 2015-03-06.

Intended use: Treatment of haemophilia A

Source: https://www.ema.europa.eu/en/medicines/human/orphan-designations/eu-3-08-551